EMA orphan designation EU/3/08/594: Strensiq
Active substance: recombinant human tissue non-specific alkaline phosphatase - Fc - deca-aspartate fusion protein
Designated 2008-12-04. Orphan designation status: Positive. EMA product number: EMEA/H/C/003794.
First published: 2009-04-24. Last updated: 2019-11-20.

Intended use: Treatment of hypophosphatasia

Source: https://www.ema.europa.eu/en/medicines/human/orphan-designations/eu-3-08-594